EMA orphan designation EU/3/21/2541
Active substance: crofelemer
Designated 2021-12-10. Orphan designation status: Positive.
First published: 2022-04-19.

Intended use: Treatment of short bowel syndrome

Source: https://www.ema.europa.eu/en/medicines/human/orphan-designations/eu-3-21-2541